EMA orphan designation EU/3/21/2450
Active substance: (S)-5-amino-3-(4-((5-fluoro-2-methoxybenzamido)methyl)phenyl)-1-(1,1,1-trifluoropropane-2-yl)-1H-pyrazole-4-carboxamide
Designated 2021-06-21. Orphan designation status: Withdrawn.
First published: 2022-05-11.

Intended use: Treatment of mantle cell lymphoma

Source: https://www.ema.europa.eu/en/medicines/human/orphan-designations/eu-3-21-2450